EMA orphan designation EU/3/18/2114
Active substance: C1 esterase inhibitor (human)
Designated 2018-12-14. Orphan designation status: Withdrawn.
First published: 2019-03-12. Last updated: 2026-05-13.

Intended use: Treatment in solid organ transplantation

Source: https://www.ema.europa.eu/en/medicines/human/orphan-designations/eu-3-18-2114